EMA orphan designation EU/3/19/2177
Active substance: regorafenib
Designated 2019-06-28. Orphan designation status: Withdrawn.
First published: 2019-10-14. Last updated: 2022-12-20.

Intended use: Treatment of glioma

Source: https://www.ema.europa.eu/en/medicines/human/orphan-designations/eu-3-19-2177